EMA orphan designation EU/3/01/021
Active substance: imatinib mesilate
Designated 2001-02-15. Orphan designation status: Expired.
First published: 2007-02-28. Last updated: 2015-02-10.

Intended use: Treatment of chronic myeloid leukaemia

Source: https://www.ema.europa.eu/en/medicines/human/orphan-designations/eu-3-01-021